EMA orphan designation EU/3/16/1673
Active substance: pyridoxine; l-pyroglutamic acid
Designated 2016-06-27. Orphan designation status: Positive.
First published: 2016-07-26. Last updated: 2016-07-26.

Intended use: Treatment of fragile X syndrome

Source: https://www.ema.europa.eu/en/medicines/human/orphan-designations/eu-3-16-1673